EMA orphan designation EU/3/07/507
Active substance: doxorubicin hydrochloride
Designated 2007-11-30. Orphan designation status: Withdrawn.
First published: 2008-07-02. Last updated: 2017-04-25.

Intended use: Treatment of glioma

Source: https://www.ema.europa.eu/en/medicines/human/orphan-designations/eu-3-07-507